EMA orphan designation EU/3/09/679
Active substance: 4-Benzyl-2-naphtalen-1-yl-1,2,4-thiadiazolidine-3,5-dione
Designated 2009-10-28. Orphan designation status: Withdrawn.
First published: 2009-11-18. Last updated: 2013-10-17.

Intended use: Treatment of progressive supranuclear palsy

Source: https://www.ema.europa.eu/en/medicines/human/orphan-designations/eu-3-09-679